EMA orphan designation EU/3/02/111: Prohippur
Active substance: benzoic acid, sodium salt
Designated 2002-09-11. Orphan designation status: Positive. EMA product number: EMEA/H/C/004150.
First published: 2003-01-08. Last updated: 2003-01-08.

Intended use: Treatment of non-ketotic hyperglycinaemia

Source: https://www.ema.europa.eu/en/medicines/human/orphan-designations/eu-3-02-111